EMA orphan designation EU/3/00/001
Active substance: somatropin
Designated 2000-08-08. Orphan designation status: Withdrawn.
First published: 2009-03-03. Last updated: 2020-10-15.

Intended use: AIDS wasting

Source: https://www.ema.europa.eu/en/medicines/human/orphan-designations/eu-3-00-001